EMA orphan designation EU/3/20/2378
Active substance: adeno-associated viral vector serotype 9 encoding human ATP7B
Designated 2020-12-09. Orphan designation status: Positive.
First published: 2021-05-27.

Intended use: Treatment of Wilson's disease

Source: https://www.ema.europa.eu/en/medicines/human/orphan-designations/eu-3-20-2378